EMA orphan designation EU/3/07/526
Active substance: N- (2-Amino-phenyl)-4-[(4-pyridin-3-yl-pyrimidin-2-ylamino)-methyl] benzamide (mocetinostat)
Designated 2008-01-31. Orphan designation status: Withdrawn.
First published: 2009-09-23. Last updated: 2022-05-04.

Intended use: Treatment of acute myeloid leukaemia

Source: https://www.ema.europa.eu/en/medicines/human/orphan-designations/eu-3-07-526